EMA orphan designation EU/3/14/1283
Active substance: mixture of two adeno-associated viral vectors of serotye 8 containing the 5'-half sequence of human ABCA4 gene and the 3'-half sequence of human ABCA4 gene
Designated 2014-07-04. Orphan designation status: Positive.
First published: 2014-07-16. Last updated: 2022-11-07.

Intended use: Treatment of Stargardt's disease

Source: https://www.ema.europa.eu/en/medicines/human/orphan-designations/eu-3-14-1283